EMA orphan designation EU/3/20/2279
Active substance: Lumacaftor
Designated 2020-06-04. Orphan designation status: Positive.
First published: 2020-08-19.

Intended use: Treatment of non-traumatic subarachnoid haemorrhage

Source: https://www.ema.europa.eu/en/medicines/human/orphan-designations/eu-3-20-2279